EMA orphan designation EU/3/20/2330
Active substance: 1-(3-methylbutanoyl)-L-aspartyl-L-threonyl-L-histidyl-L-phenylalanyl-L-prolyl-(L-cystinyl-L-isoleucyl-[(N6-(S)-4-carboxy-4-palmitamidobutanoyl)-L-lysinyl]-L-phenylalanyl-L-glutamyl-L-prolyl-L-arginyl-L-serinyl-L-lysinyl-L-glycinyl-L-cystinyl)-L-lysinamide, disulfide, acetate salt
Designated 2020-10-19. Orphan designation status: Positive.
First published: 2021-02-10.

Intended use: Treatment of polycythaemia vera

Source: https://www.ema.europa.eu/en/medicines/human/orphan-designations/eu-3-20-2330